EMA orphan designation EU/3/25/3056
Active substance: Doxorubicin, liposomal, pegylated
Designated 2025-05-22. Orphan designation status: Positive.
First published: 2025-06-25.

Intended use: Treatment of soft-tissue sarcoma

Source: https://www.ema.europa.eu/en/medicines/human/orphan-designations/eu-3-25-3056